EMA orphan designation EU/3/09/697
Active substance: N-[6-(cis-2,6-dimethylmorpholin-4-yl)pyridine-3-yl]-2-methyl-4'-(trifluoromethoxy)[1,1'-biphenyl]-3-carboxamide
Designated 2009-11-25. Orphan designation status: Withdrawn.
First published: 2009-12-10. Last updated: 2015-02-10.

Intended use: Treatment of naevoid basal-cell carcinoma syndrome (Gorlin syndrome)

Source: https://www.ema.europa.eu/en/medicines/human/orphan-designations/eu-3-09-697